EMA orphan designation EU/3/21/2416
Active substance: 5-fluoro-4-(7'-fluoro-2'-methylspiro[cyclopentane-1,3'-indol]-5'-yl)-N-(5-(1-methylpiperidin-4-yl)pyridin-2-yl)pyrimidin-2-amine; crozbaciclib
Designated 2021-03-26. Orphan designation status: Positive.
First published: 2022-01-19. Last updated: 2024-10-22.

Intended use: Treatment of glioma

Source: https://www.ema.europa.eu/en/medicines/human/orphan-designations/eu-3-21-2416